EMA orphan designation EU/3/18/2053
Active substance: tamibarotene
Designated 2018-07-31. Orphan designation status: Positive.
First published: 2018-10-10. Last updated: 2019-05-21.

Intended use: Treatment of acute myeloid leukaemia

Source: https://www.ema.europa.eu/en/medicines/human/orphan-designations/eu-3-18-2053